EMA orphan designation EU/3/12/1009: Duvyzat
Active substance: givinostat
Designated 2012-07-04. Orphan designation status: Positive. EMA product number: EMEA/H/C/006079.
First published: 2012-07-24. Last updated: 2025-03-28.

Intended use: Treatment of Duchenne muscular dystrophy

Source: https://www.ema.europa.eu/en/medicines/human/orphan-designations/eu-3-12-1009